EMA orphan designation EU/3/14/1267
Active substance: synthetic double-stranded siRNA oligonucleotide directed against transthyretin mRNA and covalently linked to a ligand containing three N-acetylgalactosamine residues for the treatment of ATTR amyloidosis
Designated 2014-04-29. Orphan designation status: Withdrawn.
First published: 2014-06-04. Last updated: 2017-05-17.

Intended use: Treatment of ATTR amyloidosis

Source: https://www.ema.europa.eu/en/medicines/human/orphan-designations/eu-3-14-1267